EMA orphan designation EU/3/23/2856
Active substance: messenger RNA encoding Cas9, single guide RNA targeting the human KLKB1 gene
Designated 2023-11-08. Orphan designation status: Positive.
First published: 2024-01-24.

Intended use: Treatment of hereditary angioedema

Source: https://www.ema.europa.eu/en/medicines/human/orphan-designations/eu-3-23-2856